EMA orphan designation EU/3/01/032
Active substance: arsenic trioxide
Designated 2001-03-29. Orphan designation status: Withdrawn.
First published: 2007-07-04. Last updated: 2010-03-15.

Intended use: Treatment of multiple myeloma

Source: https://www.ema.europa.eu/en/medicines/human/orphan-designations/eu-3-01-032